EMA orphan designation EU/3/20/2315
Active substance: pentosan polysulfate sodium
Designated 2020-08-21. Orphan designation status: Positive.
First published: 2020-11-25. Last updated: 2022-12-15.

Intended use: Treatment of mucopolysaccharidosis VI (Maroteaux-Lamy syndrome)

Source: https://www.ema.europa.eu/en/medicines/human/orphan-designations/eu-3-20-2315